EMA orphan designation EU/3/05/267
Active substance: (Z)-N-[2-(Diethylamino)ethyl]-5-[(5-fluoro-2-oxo-1,2-dihydro-3H-indol-3-ylidene)methyl]-2,4-dimethyl-1H-pyrrole-3-carboxamide (S)-2-hydroxysyccinate
Designated 2005-03-10. Orphan designation status: Withdrawn.
First published: 2009-10-15. Last updated: 2009-10-15.

Intended use: Treatment of malignant gastrointestinal stromal tumours

Source: https://www.ema.europa.eu/en/medicines/human/orphan-designations/eu-3-05-267